EMA orphan designation EU/3/05/314
Active substance: (1R,2S) 6-bromo-alpha-[2-(dimethylamino)ethyl]-2-methoxy-alpha-(1-naphthyl)-beta-phenyl-3-quinolineethanol bedaquiline
Designated 2005-08-26. Orphan designation status: Withdrawn.
First published: 2009-09-17. Last updated: 2024-03-13.

Intended use: Treatment of tuberculosis

Source: https://www.ema.europa.eu/en/medicines/human/orphan-designations/eu-3-05-314